EMA orphan designation EU/3/08/528
Active substance: Lumiliximab
Designated 2008-02-03. Orphan designation status: Withdrawn.
First published: 2008-07-10. Last updated: 2010-06-23.

Intended use: Treatment chronic lymphocytic leukaemia

Source: https://www.ema.europa.eu/en/medicines/human/orphan-designations/eu-3-08-528